EMA orphan designation EU/3/07/448
Active substance: talactoferrinum alfa
Designated 2007-06-05. Orphan designation status: Positive.
First published: 2007-07-19. Last updated: 2007-07-19.

Intended use: Treatment of renal-cell carcinoma

Source: https://www.ema.europa.eu/en/medicines/human/orphan-designations/eu-3-07-448